EMA orphan designation EU/3/05/278
Active substance: 3-[5-(2-Fluoro-phenyl)-[1,2,4]oxadiazole-3-yl]-benzoic acid (ataluren)
Designated 2005-05-27. Orphan designation status: Positive.
First published: 2007-07-04. Last updated: 2015-03-10.

Intended use: Treatment of Duchenne muscular dystrophy

Source: https://www.ema.europa.eu/en/medicines/human/orphan-designations/eu-3-05-278